EMA orphan designation EU/3/25/3044
Active substance: bexmarilimab
Designated 2025-03-25. Orphan designation status: Positive.
First published: 2025-04-04.

Intended use: Treatment of myelodysplastic syndromes

Source: https://www.ema.europa.eu/en/medicines/human/orphan-designations/eu-3-25-3044